EMA orphan designation EU/3/03/146
Active substance: aldesleukin
Designated 2003-06-30. Orphan designation status: Withdrawn.
First published: 2007-02-23. Last updated: 2007-02-23.

Intended use: Treatment of renal-cell carcinoma

Source: https://www.ema.europa.eu/en/medicines/human/orphan-designations/eu-3-03-146